EMA orphan designation EU/3/25/3026
Active substance: adeno-associated virus vector serotype SNY001 containing the human PAH gene
Designated 2025-02-26. Orphan designation status: Positive.
First published: 2025-03-11.

Intended use: Treatment of hyperphenylalaninaemia

Source: https://www.ema.europa.eu/en/medicines/human/orphan-designations/eu-3-25-3026